EMA orphan designation EU/3/09/690
Active substance: human anthrax immunoglobulin
Designated 2009-11-09. Orphan designation status: Withdrawn.
First published: 2009-11-18. Last updated: 2016-08-11.

Intended use: Post-exposure prophylaxis of inhalation anthrax disease

Source: https://www.ema.europa.eu/en/medicines/human/orphan-designations/eu-3-09-690